EMA orphan designation EU/3/20/2294
Active substance: sodium cromoglicate
Designated 2020-06-26. Orphan designation status: Withdrawn.
First published: 2020-09-23. Last updated: 2020-12-08.

Intended use: Treatment of idiopathic pulmonary fibrosis

Source: https://www.ema.europa.eu/en/medicines/human/orphan-designations/eu-3-20-2294